EMA orphan designation EU/3/11/892
Active substance: 5-[1-(2,6-Dichlorobenzyl)piperidin-4-ylmethoxy]quinazoline-2,4-diamine dihydrochloride
Designated 2011-08-30. Orphan designation status: Positive.
First published: 2011-09-21. Last updated: 2011-09-21.

Intended use: Treatment of 5q spinal muscular atrophy

Source: https://www.ema.europa.eu/en/medicines/human/orphan-designations/eu-3-11-892